EMA orphan designation EU/3/19/2136
Active substance: allogeneic cultured postnatal thymus-derived tissue
Designated 2019-02-26. Orphan designation status: Withdrawn.
First published: 2019-04-09. Last updated: 2024-04-08.

Intended use: Treatment of CHARGE syndrome

Source: https://www.ema.europa.eu/en/medicines/human/orphan-designations/eu-3-19-2136